EMA orphan designation EU/3/09/681
Active substance: 6-chloro-2,3,4,9-tetrahydro-1H-carbazole-1-carboxamide (selisistat)
Designated 2009-10-08. Orphan designation status: Positive.
First published: 2009-11-18. Last updated: 2022-02-04.

Intended use: Treatment of Huntington’s disease

Source: https://www.ema.europa.eu/en/medicines/human/orphan-designations/eu-3-09-681